EMA orphan designation EU/3/12/969
Active substance: allogeneic human dendritic cells derived from a CD34+ progenitor cell line
Designated 2012-05-22. Orphan designation status: Positive.
First published: 2012-07-06. Last updated: 2022-09-15.

Intended use: Treatment of acute myeloid leukaemia

Source: https://www.ema.europa.eu/en/medicines/human/orphan-designations/eu-3-12-969